EMA orphan designation EU/3/13/1169
Active substance: cisplatin
Designated 2013-08-05. Orphan designation status: Positive.
First published: 2013-09-12. Last updated: 2013-09-12.

Intended use: Treatment of osteosarcoma

Source: https://www.ema.europa.eu/en/medicines/human/orphan-designations/eu-3-13-1169